EMA orphan designation EU/3/16/1702
Active substance: recombinant human monoclonal antibody to insulin receptor
Designated 2016-07-14. Orphan designation status: Positive.
First published: 2016-09-06. Last updated: 2022-06-30.

Intended use: Treatment of congenital hyperinsulinism

Source: https://www.ema.europa.eu/en/medicines/human/orphan-designations/eu-3-16-1702